EMA orphan designation EU/3/23/2868
Active substance: autologous T lymphocytes containing a T-cell receptor against Epstein–Barr virus
Designated 2023-12-13. Orphan designation status: Positive.
First published: 2024-02-02.

Intended use: Treatment of peripheral T-cell lymphoma

Source: https://www.ema.europa.eu/en/medicines/human/orphan-designations/eu-3-23-2868